EMA orphan designation EU/3/17/1847
Active substance: milademetan tosilate monohydrate
Designated 2017-03-20. Orphan designation status: Positive.
First published: 2017-05-05. Last updated: 2024-10-23.

Intended use: Treatment of soft tissue sarcoma

Source: https://www.ema.europa.eu/en/medicines/human/orphan-designations/eu-3-17-1847